EMA orphan designation EU/3/15/1515
Active substance: synthetic hypericin
Designated 2015-07-28. Orphan designation status: Positive.
First published: 2015-08-18. Last updated: 2024-04-11.

Intended use: Treatment of cutaneous T-cell lymphoma

Source: https://www.ema.europa.eu/en/medicines/human/orphan-designations/eu-3-15-1515